EMA orphan designation EU/3/13/1130
Active substance: recombinant human transglutaminase 1 encapsulated into liposomes
Designated 2013-06-07. Orphan designation status: Positive.
First published: 2013-06-25. Last updated: 2013-06-25.

Intended use: Treatment of transglutaminase-1-deficient autosomal recessive congenital ichthyosis

Source: https://www.ema.europa.eu/en/medicines/human/orphan-designations/eu-3-13-1130